EMA orphan designation EU/3/06/420
Active substance: temsirolimus
Designated 2006-11-07. Orphan designation status: Expired.
First published: 2009-04-02. Last updated: 2011-11-18.

Intended use: Treatment of mantle cell lymphoma

Source: https://www.ema.europa.eu/en/medicines/human/orphan-designations/eu-3-06-420